EMA orphan designation EU/3/01/058
Active substance: repertaxin L-lysine salt
Designated 2001-09-19. Orphan designation status: Positive.
First published: 2009-05-15. Last updated: 2015-05-13.

Intended use: Prevention of delayed graft function in organ transplant

Source: https://www.ema.europa.eu/en/medicines/human/orphan-designations/eu-3-01-058